EMA orphan designation EU/3/10/827: Kanuma
Active substance: recombinant human lysosomal acid lipase
Designated 2010-12-17. Orphan designation status: Positive. EMA product number: EMEA/H/C/004004.
First published: 2011-01-20. Last updated: 2019-11-20.

Intended use: Treatment of lysosomal acid lipase deficiency

Source: https://www.ema.europa.eu/en/medicines/human/orphan-designations/eu-3-10-827